EMA orphan designation EU/3/15/1435
Active substance: nitroglycerin
Designated 2015-02-12. Orphan designation status: Positive.
First published: 2015-04-08. Last updated: 2015-04-08.

Intended use: Treatment of systemic sclerosis

Source: https://www.ema.europa.eu/en/medicines/human/orphan-designations/eu-3-15-1435